EMA orphan designation EU/3/22/2697
Active substance: Inaxaplin
Designated 2022-10-11. Orphan designation status: Positive.
First published: 2023-01-24.

Intended use: Treatment of apolipoprotein L1-mediated kidney disease

Source: https://www.ema.europa.eu/en/medicines/human/orphan-designations/eu-3-22-2697